EMA orphan designation EU/3/08/589
Active substance: yttrium (90Y) edotreotide
Designated 2008-08-04. Orphan designation status: Withdrawn.
First published: 2009-04-20. Last updated: 2018-05-29.

Intended use: Treatment of gastro-entero-pancreatic neuroendocrine tumours

Source: https://www.ema.europa.eu/en/medicines/human/orphan-designations/eu-3-08-589